EMA orphan designation EU/3/22/2579
Active substance: 18‐mer antisense oligonucleotide complementary to SCN1A mRNA; sodium salt
Designated 2022-02-24. Orphan designation status: Positive.
First published: 2022-05-13. Last updated: 2022-09-08.

Intended use: Treatment of Dravet syndrome

Source: https://www.ema.europa.eu/en/medicines/human/orphan-designations/eu-3-22-2579